EMA orphan designation EU/3/10/832
Active substance: Deferiprone
Designated 2011-02-23. Orphan designation status: Positive.
First published: 2011-03-04. Last updated: 2020-02-04.

Intended use: Treatment of sickle cell disease

Source: https://www.ema.europa.eu/en/medicines/human/orphan-designations/eu-3-10-832